EMA orphan designation EU/3/09/614
Active substance: mifepristone
Designated 2009-02-27. Orphan designation status: Positive.
First published: 2009-06-29. Last updated: 2009-06-29.

Intended use: Treatment of hypercortisolism (Cushing's syndrome) of endogenous origin

Source: https://www.ema.europa.eu/en/medicines/human/orphan-designations/eu-3-09-614